EMA orphan designation EU/3/18/2020: Darzalex
Active substance: daratumumab
Designated 2018-05-25. Orphan designation status: Positive. EMA product number: EMEA/H/C/004077.
First published: 2018-07-23. Last updated: 2021-08-03.

Intended use: Treatment of AL amyloidosis

Source: https://www.ema.europa.eu/en/medicines/human/orphan-designations/eu-3-18-2020